EMA orphan designation EU/3/16/1727
Active substance: recombinant human interleukin-12
Designated 2016-08-29. Orphan designation status: Positive.
First published: 2016-10-03. Last updated: 2018-05-03.

Intended use: Treatment of acute radiation syndrome

Source: https://www.ema.europa.eu/en/medicines/human/orphan-designations/eu-3-16-1727